EMA orphan designation EU/3/05/311
Active substance: troxacitabine
Designated 2005-08-26. Orphan designation status: Withdrawn.
First published: 2008-07-29. Last updated: 2008-07-29.

Intended use: Treatment of acute myeloid leukaemia

Source: https://www.ema.europa.eu/en/medicines/human/orphan-designations/eu-3-05-311